EMA orphan designation EU/3/04/218
Active substance: (R, S)-3-(bromomethyl)-3-butanol-1-yl-disphosphate
Designated 2004-07-29. Orphan designation status: Withdrawn.
First published: 2007-05-30. Last updated: 2010-11-18.

Intended use: Treatment of renal-cell carcinoma

Source: https://www.ema.europa.eu/en/medicines/human/orphan-designations/eu-3-04-218